EMA orphan designation EU/3/21/2559
Active substance: fasudil hydrochloride
Designated 2022-01-14. Orphan designation status: Positive.
First published: 2022-06-09.

Intended use: Treatment of amyotrophic lateral sclerosis

Source: https://www.ema.europa.eu/en/medicines/human/orphan-designations/eu-3-21-2559